EMA orphan designation EU/3/18/2046
Active substance: givinostat
Designated 2018-07-31. Orphan designation status: Positive.
First published: 2018-10-11.

Intended use: Treatment of Becker muscular dystrophy

Source: https://www.ema.europa.eu/en/medicines/human/orphan-designations/eu-3-18-2046